EMA orphan designation EU/3/14/1252
Active substance: cysteamine bitartrate (gastroresistant) (mercaptamine)
Designated 2014-03-26. Orphan designation status: Positive.
First published: 2014-05-06. Last updated: 2014-05-06.

Intended use: Treatment of pancreatic cancer

Source: https://www.ema.europa.eu/en/medicines/human/orphan-designations/eu-3-14-1252